EMA orphan designation EU/3/14/1393: Yescarta
Active substance: autologous T cells transduced with retroviral vector encoding an anti-CD19 CD28/CD3 zeta chimeric antigen receptor (axicabtagene ciloleucel)
Designated 2014-12-16. Orphan designation status: Positive. EMA product number: EMEA/H/C/004480.
First published: 2015-02-26. Last updated: 2022-12-16.

Intended use: Treatment of diffuse large B-cell lymphoma

Source: https://www.ema.europa.eu/en/medicines/human/orphan-designations/eu-3-14-1393